EMA orphan designation EU/3/06/388
Active substance: becatecarin
Designated 2006-07-25. Orphan designation status: Withdrawn.
First published: 2009-04-24. Last updated: 2019-02-21.

Intended use: Treatment of cancers of the biliary tree

Source: https://www.ema.europa.eu/en/medicines/human/orphan-designations/eu-3-06-388